EMA orphan designation EU/3/07/434
Active substance: idebenone
Designated 2007-02-16. Orphan designation status: Expired.
First published: 2008-07-10. Last updated: 2025-09-16.

Intended use: Treatment of Leber's hereditary optic neuropathy

Source: https://www.ema.europa.eu/en/medicines/human/orphan-designations/eu-3-07-434